EMA orphan designation EU/3/14/1377
Active substance: chloroquine
Designated 2014-11-19. Orphan designation status: Positive.
First published: 2015-01-16. Last updated: 2015-01-16.

Intended use: Treatment of glioma

Source: https://www.ema.europa.eu/en/medicines/human/orphan-designations/eu-3-14-1377